EMA orphan designation EU/3/23/2794
Active substance: autologous CD34+ haematopoietic stem and progenitor cells transduced with a lentiviral vector encoding the interferon alpha-2 gene
Designated 2023-06-20. Orphan designation status: Positive.
First published: 2024-02-02.

Intended use: Treatment of glioma

Source: https://www.ema.europa.eu/en/medicines/human/orphan-designations/eu-3-23-2794